EMA orphan designation EU/3/24/2956
Active substance: adeno-associated virus serotype A101 containing the codon-optimized human CFTRdeltaR
Designated 2024-07-25. Orphan designation status: Positive.
First published: 2024-09-13.

Intended use: Treatment of cystic fibrosis

Source: https://www.ema.europa.eu/en/medicines/human/orphan-designations/eu-3-24-2956